EMA orphan designation EU/3/04/250
Active substance: recombinant histidine-tagged idiotype immunoglobulin Fab fragment of clonal B-cell receptors
Designated 2004-12-21. Orphan designation status: Positive.
First published: 2006-01-06. Last updated: 2011-06-01.

Intended use: Treatment of mantle cell lymphoma

Source: https://www.ema.europa.eu/en/medicines/human/orphan-designations/eu-3-04-250